EMA orphan designation EU/3/09/658
Active substance: tamibarotene
Designated 2009-07-24. Orphan designation status: Withdrawn.
First published: 2009-09-11. Last updated: 2013-07-31.

Intended use: Treatment of acute promyelocytic leukaemia

Source: https://www.ema.europa.eu/en/medicines/human/orphan-designations/eu-3-09-658